EMA orphan designation EU/3/23/2791
Active substance: 5-(3,4-Dichloro-phenyl)-N-((1R,2R)-2-hydroxy-cyclohexyl)-6-(2,2,2-trifluoro-ethoxy)-nicotinamide
Designated 2023-06-20. Orphan designation status: Positive.
First published: 2024-01-30.

Intended use: Treatment of Alport syndrome

Source: https://www.ema.europa.eu/en/medicines/human/orphan-designations/eu-3-23-2791